EMA orphan designation EU/3/03/150
Active substance: adenovirus-interferon gamma-coding DNA sequence
Designated 2003-07-09. Orphan designation status: Withdrawn.
First published: 2003-10-23. Last updated: 2010-11-18.

Intended use: Treatment of cutaneous T-cell lymphoma

Source: https://www.ema.europa.eu/en/medicines/human/orphan-designations/eu-3-03-150